EMA orphan designation EU/3/22/2713
Active substance: propranolol
Designated 2022-11-11. Orphan designation status: Positive.
First published: 2023-01-23.

Intended use: Treatment of familial cerebral cavernous malformations

Source: https://www.ema.europa.eu/en/medicines/human/orphan-designations/eu-3-22-2713